EMA orphan designation EU/3/19/2237
Active substance: 2-(3,7-Dimethyl-octa-2, 6-dienyl)-6-ethylamino-3-hydroxy-5-pentyl-[1,4]benzoquinone
Designated 2020-01-09. Orphan designation status: Positive.
First published: 2020-05-15.

Intended use: Treatment of Huntington's disease

Source: https://www.ema.europa.eu/en/medicines/human/orphan-designations/eu-3-19-2237